EMA orphan designation EU/3/18/1996
Active substance: melatonin
Designated 2018-03-21. Orphan designation status: Positive.
First published: 2018-05-15. Last updated: 2017-10-23.

Intended use: Treatment of neonatal encephalopathy

Source: https://www.ema.europa.eu/en/medicines/human/orphan-designations/eu-3-18-1996